EMA orphan designation EU/3/21/2472
Active substance: mRNA encoding the human glycogen debranching enzyme
Designated 2021-07-19. Orphan designation status: Positive.
First published: 2021-11-16.

Intended use: Treatment of glycogen storage disease type III

Source: https://www.ema.europa.eu/en/medicines/human/orphan-designations/eu-3-21-2472